EMA orphan designation EU/3/21/2509
Active substance: selinexor
Designated 2021-10-15. Orphan designation status: Positive.
First published: 2022-01-21. Last updated: 2026-05-11.

Intended use: Treatment of glioma

Source: https://www.ema.europa.eu/en/medicines/human/orphan-designations/eu-3-21-2509